EMA orphan designation EU/3/19/2195
Active substance: 1-(2,2-diphenyltetrahydrofuran-3-yl)-N,N-dimethylmethanamine hydrochloride
Designated 2019-08-21. Orphan designation status: Positive.
First published: 2020-01-16.

Intended use: Treatment of Rett syndrome

Source: https://www.ema.europa.eu/en/medicines/human/orphan-designations/eu-3-19-2195